EMA orphan designation EU/3/13/1103: Trepulmix
Active substance: treprostinil sodium
Designated 2013-02-08. Orphan designation status: Positive. EMA product number: EMEA/H/C/005207.
First published: 2013-03-04. Last updated: 2020-04-08.

Intended use: Treatment of chronic thromboembolic pulmonary hypertension

Source: https://www.ema.europa.eu/en/medicines/human/orphan-designations/eu-3-13-1103